EMA orphan designation EU/3/09/621
Active substance: 2,2-Dimethylbutyric acid, sodium salt
Designated 2009-03-18. Orphan designation status: Positive.
First published: 2009-04-08. Last updated: 2015-03-20.

Intended use: Treatment of sickle cell disease

Source: https://www.ema.europa.eu/en/medicines/human/orphan-designations/eu-3-09-621